EMA orphan designation EU/3/20/2278
Active substance: rilzabrutinib
Designated 2020-06-04. Orphan designation status: Positive.
First published: 2020-08-19. Last updated: 2023-07-18.

Intended use: Treatment of immune thrombocytopenia

Source: https://www.ema.europa.eu/en/medicines/human/orphan-designations/eu-3-20-2278